EMA orphan designation EU/3/18/2067
Active substance: recombinant human monoclonal antibody against mannan-binding lectin-associated serine protease-2
Designated 2018-08-24. Orphan designation status: Positive.
First published: 2018-12-19.

Intended use: Treatment in haematopoietic stem cell transplantation

Source: https://www.ema.europa.eu/en/medicines/human/orphan-designations/eu-3-18-2067